EMA orphan designation EU/3/13/1162
Active substance: heterologous human adult liver-derived progenitor cells
Designated 2013-07-17. Orphan designation status: Positive.
First published: 2013-08-21. Last updated: 2024-10-15.

Intended use: Treatment of citrullinaemia type 1

Source: https://www.ema.europa.eu/en/medicines/human/orphan-designations/eu-3-13-1162